EMA orphan designation EU/3/06/389
Active substance: Lestaurtinib
Designated 2006-07-25. Orphan designation status: Withdrawn.
First published: 2009-02-24. Last updated: 2015-05-08.

Intended use: Treatment of acute myeloid leukaemia

Source: https://www.ema.europa.eu/en/medicines/human/orphan-designations/eu-3-06-389